EMA orphan designation EU/3/01/071
Active substance: stiripentol
Designated 2001-12-06. Orphan designation status: Expired.
First published: 2007-07-31. Last updated: 2017-02-08.

Intended use: Treatment of severe myoclonic epilepsy in infancy

Source: https://www.ema.europa.eu/en/medicines/human/orphan-designations/eu-3-01-071